EMA orphan designation EU/3/11/891
Active substance: human anthrax monoclonal antibody
Designated 2011-08-05. Orphan designation status: Withdrawn.
First published: 2011-08-16. Last updated: 2016-08-11.

Intended use: For post-exposure prophylaxis of inhalation anthrax disease

Source: https://www.ema.europa.eu/en/medicines/human/orphan-designations/eu-3-11-891